EMA orphan designation EU/3/14/1424: Minjuvi
Active substance: Humanised Fc engineered monoclonal antibody against CD19 (tafasitamab)
Designated 2015-01-15. Orphan designation status: Positive. EMA product number: EMEA/H/C/005436.
First published: 2015-03-06. Last updated: 2021-09-08.

Intended use: Treatment of diffuse large B-cell lymphoma

Source: https://www.ema.europa.eu/en/medicines/human/orphan-designations/eu-3-14-1424